EMA orphan designation EU/3/25/3053
Active substance: tertomotide
Designated 2025-05-22. Orphan designation status: Positive.
First published: 2025-06-24.

Intended use: Treatment of progressive supranuclear palsy

Source: https://www.ema.europa.eu/en/medicines/human/orphan-designations/eu-3-25-3053